EMA orphan designation EU/3/16/1680: Enspryng
Active substance: Humanised anti-IL-6 receptor monoclonal antibody (satralizumab)
Designated 2016-06-27. Orphan designation status: Positive. EMA product number: EMEA/H/C/004788.
First published: 2016-07-26. Last updated: 2021-06-30.

Intended use: Treatment of neuromyelitis optica spectrum disorders

Source: https://www.ema.europa.eu/en/medicines/human/orphan-designations/eu-3-16-1680